EMA orphan designation EU/3/18/2021
Active substance: Equine immunoglobulin F(ab')2 fragments targeting Shiga toxin
Designated 2018-05-25. Orphan designation status: Positive.
First published: 2018-07-24. Last updated: 2018-07-24.

Intended use: Prevention of haemolytic uraemic syndrome

Source: https://www.ema.europa.eu/en/medicines/human/orphan-designations/eu-3-18-2021